EMA orphan designation EU/3/10/761
Active substance: 3-(6-(1-(2,2-Difluorobenzo [d] [1,3] dioxol-5-yl)cyclopropanecarboxamido)-3-methylpyridin-2-yl)benzoic acid (lumacaftor)
Designated 2010-08-04. Orphan designation status: Withdrawn.
First published: 2010-08-11. Last updated: 2017-07-17.

Intended use: Treatment of cystic fibrosis

Source: https://www.ema.europa.eu/en/medicines/human/orphan-designations/eu-3-10-761